EMA orphan designation EU/3/16/1682
Active substance: melatonin
Designated 2016-08-01. Orphan designation status: Positive.
First published: 2016-08-02. Last updated: 2018-10-23.

Intended use: Treatment of necrotising enterocolitis

Source: https://www.ema.europa.eu/en/medicines/human/orphan-designations/eu-3-16-1682